EMA orphan designation EU/3/01/054
Active substance: sinapultide; Dipalmitoylphosphatidylcholine; palmitoyloleoyl phosphatidylglycerol; palmitic acid
Designated 2001-09-19. Orphan designation status: Withdrawn.
First published: 2007-05-30. Last updated: 2010-06-23.

Intended use: Treatment of meconium aspiration syndrome

Source: https://www.ema.europa.eu/en/medicines/human/orphan-designations/eu-3-01-054